EMA orphan designation EU/3/18/2113
Active substance: bromelain
Designated 2018-12-14. Orphan designation status: Positive.
First published: 2019-03-08. Last updated: 2020-02-05.

Intended use: Treatment of pseudomyxoma peritonei

Source: https://www.ema.europa.eu/en/medicines/human/orphan-designations/eu-3-18-2113